EMA orphan designation EU/3/05/324: Elmisol
Active substance: levamisole hydrochloride
Designated 2005-10-28. Orphan designation status: Positive. EMA product number: EMEA/H/C/004330.
First published: 2006-08-08. Last updated: 2006-08-08.

Intended use: Treatment of nephrotic syndrome

Source: https://www.ema.europa.eu/en/medicines/human/orphan-designations/eu-3-05-324